EMA orphan designation EU/3/04/201
Active substance: vascular endothelial growth factor-D gene in an adenoviral vector for use with a collagen collar
Designated 2004-06-08. Orphan designation status: Positive.
First published: 2004-12-06. Last updated: 2014-04-03.

Intended use: Prevention of stenosis in synthetic grafts used in haemodialysis

Source: https://www.ema.europa.eu/en/medicines/human/orphan-designations/eu-3-04-201